EMA orphan designation EU/3/02/086
Active substance: porfimer sodium
Designated 2002-03-06. Orphan designation status: Withdrawn.
First published: 2007-02-28. Last updated: 2013-06-20.

Intended use: Treatment of high-grade dysplasia in Barrett's oesophagus

Source: https://www.ema.europa.eu/en/medicines/human/orphan-designations/eu-3-02-086